EMA orphan designation EU/3/02/121
Active substance: 5-aminolevulinic acid hydrochloride
Designated 2002-11-14. Orphan designation status: Expired.
First published: 2007-12-04. Last updated: 2017-10-03.

Intended use: Intra-operative photodynamic diagnosis of residual glioma

Source: https://www.ema.europa.eu/en/medicines/human/orphan-designations/eu-3-02-121